EMA orphan designation EU/3/09/662
Active substance: 26 base single stranded phosphodiester DNA oligonucleotide
Designated 2009-10-08. Orphan designation status: Withdrawn.
First published: 2009-10-20. Last updated: 2011-05-18.

Intended use: Treatment of acute myeloid leukaemia

Source: https://www.ema.europa.eu/en/medicines/human/orphan-designations/eu-3-09-662